EMA orphan designation EU/3/12/1088
Active substance: allogeneic motor neuron progenitor cells derived from human embryonic stem cells
Designated 2013-01-24. Orphan designation status: Positive.
First published: 2013-03-01. Last updated: 2013-03-01.

Intended use: Treatment of 5q spinal muscular atrophy

Source: https://www.ema.europa.eu/en/medicines/human/orphan-designations/eu-3-12-1088